EMA orphan designation EU/3/07/470: Heparesc
Active substance: human heterologous liver cells
Designated 2007-09-14. Orphan designation status: Positive. EMA product number: EMEA/H/C/003750.
First published: 2008-01-22. Last updated: 2016-08-16.

Intended use: Treatment of ornithine-transcarbamylase deficiency

Source: https://www.ema.europa.eu/en/medicines/human/orphan-designations/eu-3-07-470